EMA orphan designation EU/3/09/615
Active substance: N-terminal hexaglutamine-tagged recombinant human N-acetylgalactosamine-6-sulfate sulfatase
Designated 2009-02-27. Orphan designation status: Positive.
First published: 2009-10-30. Last updated: 2022-03-18.

Intended use: Treatment of mucopolysaccharidosis, type IVA (Morquio A syndrome)

Source: https://www.ema.europa.eu/en/medicines/human/orphan-designations/eu-3-09-615